EMA orphan designation EU/3/05/345
Active substance: Lentiviral vector containing the human Wiskott Aldrich Syndrome Protein gene
Designated 2006-01-24. Orphan designation status: Withdrawn.
First published: 2009-04-24. Last updated: 2014-06-19.

Intended use: Treatment of Wiskott-Aldrich syndrome

Source: https://www.ema.europa.eu/en/medicines/human/orphan-designations/eu-3-05-345